EMA orphan designation EU/3/14/1256
Active substance: adeno-associated viral vector serotype 8 containing the human GUCY2D gene
Designated 2014-03-26. Orphan designation status: Positive.
First published: 2014-05-07. Last updated: 2022-11-07.

Intended use: Treatment of Leber's congenital amaurosis

Source: https://www.ema.europa.eu/en/medicines/human/orphan-designations/eu-3-14-1256